EMA orphan designation EU/3/07/513: Dovprela (previously Pretomanid FGK)
Active substance: (S)-2-nitro-6-(4-trifluoromethoxy)benzyloxy)-6,7-dihydro-5H-imidazo[2,1-b] [1,3] oxazine (pretomanid)
Designated 2007-11-29. Orphan designation status: Positive. EMA product number: EMEA/H/C/005167.
First published: 2008-07-02. Last updated: 2020-12-09.

Intended use: Treatment of tuberculosis

Source: https://www.ema.europa.eu/en/medicines/human/orphan-designations/eu-3-07-513